EMA orphan designation EU/3/14/1238
Active substance: Eculizumab
Designated 2014-02-19. Orphan designation status: Withdrawn.
First published: 2014-04-01. Last updated: 2017-05-17.

Intended use: Prevention of delayed graft function after solid organ transplantation

Source: https://www.ema.europa.eu/en/medicines/human/orphan-designations/eu-3-14-1238